EMA orphan designation EU/3/16/1792
Active substance: valproic acid
Designated 2016-11-18. Orphan designation status: Positive.
First published: 2016-12-14. Last updated: 2016-12-14.

Intended use: Treatment of diffuse large B-cell lymphoma

Source: https://www.ema.europa.eu/en/medicines/human/orphan-designations/eu-3-16-1792